EMA orphan designation EU/3/21/2448
Active substance: allogenic placenta-derived mesenchymal stromal cells secretome
Designated 2021-06-21. Orphan designation status: Positive.
First published: 2022-05-11.

Intended use: Treatment of pre-eclampsia

Source: https://www.ema.europa.eu/en/medicines/human/orphan-designations/eu-3-21-2448